EMA orphan designation EU/3/15/1473
Active substance: lenalidomide
Designated 2015-04-24. Orphan designation status: Withdrawn.
First published: 2015-05-21. Last updated: 2020-01-13.

Intended use: Treatment of marginal zone lymphoma

Source: https://www.ema.europa.eu/en/medicines/human/orphan-designations/eu-3-15-1473